EMA orphan designation EU/3/11/919
Active substance: clonidine hydrochloride
Designated 2011-10-27. Orphan designation status: Positive.
First published: 2011-11-23. Last updated: 2015-03-12.

Intended use: Prevention of oral mucositis in head and neck cancer patients undergoing radiation therapy

Source: https://www.ema.europa.eu/en/medicines/human/orphan-designations/eu-3-11-919